EMA orphan designation EU/3/12/1046
Active substance: Mavoglurant
Designated 2012-10-10. Orphan designation status: Withdrawn.
First published: 2012-11-16. Last updated: 2026-05-26.

Intended use: Treatment of fragile X syndrome

Source: https://www.ema.europa.eu/en/medicines/human/orphan-designations/eu-3-12-1046